EMA orphan designation EU/3/25/3113
Active substance: acetylcysteine amide
Designated 2025-08-22. Orphan designation status: Positive.
First published: 2026-04-10.

Intended use: Treatment of hereditary cerebral amyloid angiopathies

Source: https://www.ema.europa.eu/en/medicines/human/orphan-designations/eu-3-25-3113